EMA orphan designation EU/3/13/1123
Active substance: nintedanib
Designated 2013-04-26. Orphan designation status: Withdrawn.
First published: 2013-05-14. Last updated: 2020-06-09.

Intended use: Treatment of idiopathic pulmonary fibrosis

Source: https://www.ema.europa.eu/en/medicines/human/orphan-designations/eu-3-13-1123